EMA orphan designation EU/3/24/2906
Active substance: zatolmilast
Designated 2024-03-21. Orphan designation status: Positive.
First published: 2024-07-23.

Intended use: Treatment of fragile X syndrome

Source: https://www.ema.europa.eu/en/medicines/human/orphan-designations/eu-3-24-2906